EMA orphan designation EU/3/15/1451
Active substance: 5'-ASCSASTSCSASGSTSCSTSGSASUSASASGSCSTSA-3' (lademirsen)
Designated 2015-03-19. Orphan designation status: Withdrawn.
First published: 2015-05-11. Last updated: 2023-06-05.

Intended use: Treatment of Alport syndrome

Source: https://www.ema.europa.eu/en/medicines/human/orphan-designations/eu-3-15-1451